EMA orphan designation EU/3/23/2883
Active substance: cutamesine
Designated 2024-01-12. Orphan designation status: Positive.
First published: 2024-04-11.

Intended use: alpha-thalassaemia X-linked intellectual disability syndrome (due to mutations in the ATRX gene)

Source: https://www.ema.europa.eu/en/medicines/human/orphan-designations/eu-3-23-2883